EMA orphan designation EU/3/06/366
Active substance: tobramycin
Designated 2006-04-11. Orphan designation status: Withdrawn.
First published: 2009-05-15. Last updated: 2013-03-14.

Intended use: Treatment of Pseudomonas aeruginosa lung infection in cystic fibrosis

Source: https://www.ema.europa.eu/en/medicines/human/orphan-designations/eu-3-06-366